EMA orphan designation EU/3/20/2310
Active substance: Adeno-associated virus serotype 2/8 vector containing the human PDE6A gene
Designated 2020-08-21. Orphan designation status: Positive.
First published: 2020-11-25.

Intended use: Treatment of retinitis pigmentosa

Source: https://www.ema.europa.eu/en/medicines/human/orphan-designations/eu-3-20-2310